EMA orphan designation EU/3/23/2803
Active substance: Ilginatinib maleate
Designated 2023-07-25. Orphan designation status: Withdrawn.
First published: 2024-01-31. Last updated: 2026-05-26.

Intended use: Treatment of myelofibrosis

Source: https://www.ema.europa.eu/en/medicines/human/orphan-designations/eu-3-23-2803